EMA orphan designation EU/3/13/1224
Active substance: sodium nitrite
Designated 2014-01-16. Orphan designation status: Positive.
First published: 2014-03-21. Last updated: 2019-08-19.

Intended use: Treatment of aneurysmal subarachnoid haemorrhage

Source: https://www.ema.europa.eu/en/medicines/human/orphan-designations/eu-3-13-1224